EMA orphan designation EU/3/10/818: Heparesc
Active substance: human heterologous liver cells
Orphan designation status: Positive. EMA product number: EMEA/H/C/003750.
First published: 2011-01-19. Last updated: 2016-08-16.

Intended use: Treatment of citrullinaemia type 1

Source: https://www.ema.europa.eu/en/medicines/human/orphan-designations/eu-3-10-818